EMA orphan designation EU/3/04/256
Active substance: 17-allylamino-17-demethoxygeldanamycin
Designated 2005-01-26. Orphan designation status: Withdrawn.
First published: 2009-09-17. Last updated: 2009-09-17.

Intended use: Treatment of chronic myeloid leukaemia

Source: https://www.ema.europa.eu/en/medicines/human/orphan-designations/eu-3-04-256